EMA orphan designation EU/3/14/1342
Active substance: Glucagon
Orphan designation status: Positive.
First published: 2014-11-12. Last updated: 2023-06-09.

Intended use: Treatment of congenital hyperinsulinism

Source: https://www.ema.europa.eu/en/medicines/human/orphan-designations/eu-3-14-1342